EMA orphan designation EU/3/16/1710
Active substance: triheptanoin
Designated 2016-07-14. Orphan designation status: Positive.
First published: 2016-09-06. Last updated: 2016-09-06.

Intended use: Treatment of McArdle's disease

Source: https://www.ema.europa.eu/en/medicines/human/orphan-designations/eu-3-16-1710